EMA orphan designation EU/3/16/1635
Active substance: N-acetyl-D-mannosamine monohydrate
Designated 2016-03-21. Orphan designation status: Positive.
First published: 2016-05-04. Last updated: 2016-05-04.

Intended use: Treatment of GNE myopathy

Source: https://www.ema.europa.eu/en/medicines/human/orphan-designations/eu-3-16-1635